EMA orphan designation EU/3/20/2251: Artesunate Amivas
Active substance: artesunate
Designated 2020-02-28. Orphan designation status: Positive. EMA product number: EMEA/H/C/005550.
First published: 2020-05-06. Last updated: 2022-01-13.

Intended use: Treatment of malaria

Source: https://www.ema.europa.eu/en/medicines/human/orphan-designations/eu-3-20-2251